EMA orphan designation EU/3/01/063
Active substance: Deoxyribose phosphorothioate (5'-tct-ccc-agc-gtg-cgc-cat-3')
Designated 2001-11-21. Orphan designation status: Withdrawn.
First published: 2005-01-06. Last updated: 2013-04-09.

Intended use: Treatment of chronic lymphocytic leukaemia

Source: https://www.ema.europa.eu/en/medicines/human/orphan-designations/eu-3-01-063